EMA orphan designation EU/3/25/3134
Active substance: sonpiretigene isteparvovec
Designated 2025-08-22. Orphan designation status: Positive.
First published: 2026-04-10.

Intended use: Treatment of non-syndromic macular dystrophy

Source: https://www.ema.europa.eu/en/medicines/human/orphan-designations/eu-3-25-3134